EMA orphan designation EU/3/17/1852
Active substance: allogeneic ex-vivo-expanded umbilical cord blood-derived haematopoietic CD34+ progenitor cells and allogeneic non-expanded umbilical cord blood-derived haematopoietic mature myeloid and lymphoid cells
Designated 2017-03-20. Orphan designation status: Positive.
First published: 2017-05-05. Last updated: 2021-10-13.

Intended use: Treatment in haematopoietic stem cell transplantation

Source: https://www.ema.europa.eu/en/medicines/human/orphan-designations/eu-3-17-1852